EMA orphan designation EU/3/23/2851
Active substance: N-{(2S,3R)-4,4-Difluoro-1-(2-hydroxy-2-methylpropanoyl)-2-[(2,3',5'-trifluoro[1,1'-biphenyl]-3-yl)methyl]pyrrolidin-3-yl}ethanesulfonamide
Designated 2023-11-08. Orphan designation status: Positive.
First published: 2024-01-31.

Intended use: Treatment of narcolepsy

Source: https://www.ema.europa.eu/en/medicines/human/orphan-designations/eu-3-23-2851